EMA orphan designation EU/3/15/1518: Luxturna
Active substance: adenovirus-associated viral vector serotype 2 containing the human RPE65 gene (voretigene neparvovec)
Designated 2015-07-28. Orphan designation status: Positive. EMA product number: EMEA/H/C/004451.
First published: 2015-08-18. Last updated: 2019-03-04.

Intended use: Treatment of retinitis pigmentosa

Source: https://www.ema.europa.eu/en/medicines/human/orphan-designations/eu-3-15-1518